EMA orphan designation EU/3/23/2831
Active substance: sildenafil citrate
Designated 2023-10-13. Orphan designation status: Positive.
First published: 2024-02-01.

Intended use: Treatment of Leigh syndrome

Source: https://www.ema.europa.eu/en/medicines/human/orphan-designations/eu-3-23-2831